EMA orphan designation EU/3/13/1208
Active substance: soraprazan
Designated 2013-11-13. Orphan designation status: Positive.
First published: 2013-12-02. Last updated: 2013-12-02.

Intended use: Treatment of Stargardt's disease

Source: https://www.ema.europa.eu/en/medicines/human/orphan-designations/eu-3-13-1208